EMA orphan designation EU/3/20/2365
Active substance: adeno-associated virus serotype rh74 containing the human sarcoglycan beta gene
Designated 2020-12-09. Orphan designation status: Positive.
First published: 2021-05-19.

Intended use: Treatment of limb-girdle muscular dystrophy

Source: https://www.ema.europa.eu/en/medicines/human/orphan-designations/eu-3-20-2365